EMA orphan designation EU/3/10/791
Active substance: ciclosporin
Designated 2010-10-01. Orphan designation status: Positive.
First published: 2010-10-14. Last updated: 2021-02-03.

Intended use: Treatment of moderate and severe closed traumatic brain injury

Source: https://www.ema.europa.eu/en/medicines/human/orphan-designations/eu-3-10-791